EMA orphan designation EU/3/21/2568
Active substance: azithromycin dihydrate
Designated 2022-01-14. Orphan designation status: Positive.
First published: 2022-06-15. Last updated: 2024-04-08.

Intended use: Prevention of bronchopulmonary dysplasia

Source: https://www.ema.europa.eu/en/medicines/human/orphan-designations/eu-3-21-2568